EMA orphan designation EU/3/16/1812
Active substance: antroquinonol
Designated 2017-01-12. Orphan designation status: Positive.
First published: 2017-07-14. Last updated: 2017-07-14.

Intended use: Treatment of pancreatic cancer

Source: https://www.ema.europa.eu/en/medicines/human/orphan-designations/eu-3-16-1812